EMA orphan designation EU/3/16/1698
Active substance: dimethyl fumarate
Designated 2016-07-14. Orphan designation status: Positive.
First published: 2016-09-06. Last updated: 2020-03-02.

Intended use: Treatment of bullous pemphigoid

Source: https://www.ema.europa.eu/en/medicines/human/orphan-designations/eu-3-16-1698